EMA orphan designation EU/3/14/1427
Active substance: recombinant human alkaline phosphatase
Designated 2015-01-15. Orphan designation status: Positive.
First published: 2015-03-06. Last updated: 2015-03-06.

Intended use: Treatment of hypophosphatasia

Source: https://www.ema.europa.eu/en/medicines/human/orphan-designations/eu-3-14-1427